EMA orphan designation EU/3/22/2653
Active substance: efgartigimod alfa
Designated 2022-07-18. Orphan designation status: Withdrawn.
First published: 2023-01-23.

Intended use: Treatment of pemphigus

Source: https://www.ema.europa.eu/en/medicines/human/orphan-designations/eu-3-22-2653